EMA orphan designation EU/3/21/2529
Active substance: soticlestat
Designated 2021-11-12. Orphan designation status: Positive.
First published: 2022-06-09. Last updated: 2024-10-23.

Intended use: Treatment of Lennox-Gastaut syndrome

Source: https://www.ema.europa.eu/en/medicines/human/orphan-designations/eu-3-21-2529